EMA orphan designation EU/3/25/3121
Active substance: brepocitinib
Designated 2025-08-22. Orphan designation status: Positive.
First published: 2026-04-10.

Intended use: Treatment of idiopathic inflammatory myopathy

Source: https://www.ema.europa.eu/en/medicines/human/orphan-designations/eu-3-25-3121